EMA orphan designation EU/3/17/1955
Active substance: adeno-associated viral vector serotype 2/6 encoding zinc-finger nucleases and the human alpha L-iduronidase gene (devafidugene civaparvovec)
Designated 2018-01-17. Orphan designation status: Withdrawn.
First published: 2018-03-12. Last updated: 2023-09-12.

Intended use: Treatment of mucopolysaccharidosis type I

Source: https://www.ema.europa.eu/en/medicines/human/orphan-designations/eu-3-17-1955